EMA orphan designation EU/3/24/2892
Designated 2024-02-19. Orphan designation status: Positive.
First published: 2024-04-11.

Intended use: Treatment of small cell lung cancer

Source: https://www.ema.europa.eu/en/medicines/human/orphan-designations/eu-3-24-2892